EMA orphan designation EU/3/22/2716: Aspaveli
Active substance: pegcetacoplan
Designated 2022-11-11. Orphan designation status: Positive. EMA product number: EMEA/H/C/005553.
First published: 2023-01-23.

Intended use: Treatment of C3 glomerulopathy with or without immune complexes

Source: https://www.ema.europa.eu/en/medicines/human/orphan-designations/eu-3-22-2716